EMA orphan designation EU/3/07/495
Active substance: methotrexate
Designated 2007-10-24. Orphan designation status: Positive.
First published: 2008-07-02. Last updated: 2014-07-11.

Intended use: Treatment of acute lymphoblastic leukaemia

Source: https://www.ema.europa.eu/en/medicines/human/orphan-designations/eu-3-07-495